EMA orphan designation EU/3/19/2204
Active substance: 18-(p-(131I)-iodophenyl)octadecyl phosphocholine
Designated 2019-10-17. Orphan designation status: Positive.
First published: 2020-01-21. Last updated: 2021-05-11.

Intended use: Treatment of multiple myeloma

Source: https://www.ema.europa.eu/en/medicines/human/orphan-designations/eu-3-19-2204